EMA orphan designation EU/3/18/2063
Active substance: CD34+ haematopoietic stem and progenitor cells with CD3+ T-cells
Designated 2018-08-24. Orphan designation status: Positive.
First published: 2018-12-20. Last updated: 2021-11-30.

Intended use: Treatment in solid organ transplantation

Source: https://www.ema.europa.eu/en/medicines/human/orphan-designations/eu-3-18-2063